EMA orphan designation EU/3/16/1654
Active substance: S-acetyl-(S)-4'-phosphopantetheine, calcium salt
Designated 2016-04-28. Orphan designation status: Positive.
First published: 2016-05-31. Last updated: 2019-12-02.

Intended use: Treatment of pantothenate-kinase-associated neurodegeneration

Source: https://www.ema.europa.eu/en/medicines/human/orphan-designations/eu-3-16-1654